EMA orphan designation EU/3/14/1326
Active substance: vector based on an adeno-associated virus serotype 2 backbone, pseudo-serotyped with a type 8 capsid, which carries the coding sequence of the human TYMP gene under the control of the human thyroxine binding globulin promoter
Designated 2014-08-22. Orphan designation status: Positive.
First published: 2014-10-02. Last updated: 2014-10-02.

Intended use: Treatment of mitochondrial neurogastrointestinal encephalomyopathy (MNGIE)

Source: https://www.ema.europa.eu/en/medicines/human/orphan-designations/eu-3-14-1326